EMA orphan designation EU/3/11/901
Active substance: Dinaciclib
Designated 2011-09-27. Orphan designation status: Withdrawn.
First published: 2011-10-17. Last updated: 2022-02-25.

Intended use: Treatment of chronic lymphocytic leukaemia

Source: https://www.ema.europa.eu/en/medicines/human/orphan-designations/eu-3-11-901